EMA orphan designation EU/3/08/588: Adzynma
Active substance: recombinant human ADAMTS-13
Designated 2008-12-03. Orphan designation status: Positive. EMA product number: EMEA/H/C/006198.
First published: 2009-06-29. Last updated: 2024-08-01.

Intended use: Treatment of thrombotic thrombocytopenic purpura

Source: https://www.ema.europa.eu/en/medicines/human/orphan-designations/eu-3-08-588